EMA orphan designation EU/3/12/1076
Active substance: allopurinol sodium
Designated 2012-12-06. Orphan designation status: Positive.
First published: 2013-01-25. Last updated: 2016-05-23.

Intended use: Treatment of perinatal asphyxia

Source: https://www.ema.europa.eu/en/medicines/human/orphan-designations/eu-3-12-1076